EMA orphan designation EU/3/14/1387
Active substance: Exisulind
Designated 2014-12-16. Orphan designation status: Positive.
First published: 2015-02-26. Last updated: 2015-02-26.

Intended use: Treatment of familial cerebral cavernous malformations

Source: https://www.ema.europa.eu/en/medicines/human/orphan-designations/eu-3-14-1387